EMA orphan designation EU/3/03/171
Active substance: trabectedin
Designated 2003-10-17. Orphan designation status: Expired.
First published: 2004-10-01. Last updated: 2019-11-27.

Intended use: Treatment of ovarian cancer

Source: https://www.ema.europa.eu/en/medicines/human/orphan-designations/eu-3-03-171